EMA orphan designation EU/3/15/1583
Active substance: 2-amino-2-[2-[2-chloro-4-[[3-(phenylmethoxy)phenyl]thio]phenyl]ethyl]-1,3-propanediol hydrochloride
Designated 2015-12-14. Orphan designation status: Withdrawn.
First published: 2016-02-02. Last updated: 2018-01-22.

Intended use: Prevention of graft-versus-host disease

Source: https://www.ema.europa.eu/en/medicines/human/orphan-designations/eu-3-15-1583